EMA orphan designation EU/3/22/2576
Active substance: Gadolinium-chelated polysiloxane nanoparticles
Designated 2022-02-24. Orphan designation status: Positive.
First published: 2022-04-29.

Intended use: Treatment of pancreatic cancer

Source: https://www.ema.europa.eu/en/medicines/human/orphan-designations/eu-3-22-2576